EMA orphan designation EU/3/20/2377
Active substance: 2’-O-(2-methoxyethyl) modified antisense oligonucleotide targeting glycogen synthase 1 pre‑mRNA
Designated 2020-12-09. Orphan designation status: Positive.
First published: 2021-05-27.

Intended use: Treatment of progressive myoclonic epilepsy type 2 (Lafora disease)

Source: https://www.ema.europa.eu/en/medicines/human/orphan-designations/eu-3-20-2377